EMA orphan designation EU/3/17/1886
Active substance: sirolimus
Designated 2017-06-20. Orphan designation status: Positive.
First published: 2017-07-17. Last updated: 2022-07-19.

Intended use: Treatment of tuberous sclerosis

Source: https://www.ema.europa.eu/en/medicines/human/orphan-designations/eu-3-17-1886